EMA orphan designation EU/3/14/1405
Active substance: autologous collagen type II-specific regulatory T cells
Designated 2014-12-16. Orphan designation status: Withdrawn.
First published: 2015-02-27. Last updated: 2019-11-19.

Intended use: Treatment of non-infectious uveitis

Source: https://www.ema.europa.eu/en/medicines/human/orphan-designations/eu-3-14-1405